EMA orphan designation EU/3/23/2866
Active substance: marstacimab
Designated 2023-12-13. Orphan designation status: Withdrawn.
First published: 2024-02-01. Last updated: 2024-11-19.

Intended use: Treatment of haemophilia B

Source: https://www.ema.europa.eu/en/medicines/human/orphan-designations/eu-3-23-2866